EMA orphan designation EU/3/18/2049
Active substance: recombinant human ectonucleotide pyrophosphatase/phosphodiesterase 1 fused to the Fc fragment of IgG1
Designated 2018-07-31. Orphan designation status: Positive.
First published: 2018-10-11.

Intended use: treatment of ectonucleotide pyrophosphatase/phosphodiesterase 1 deficiency

Source: https://www.ema.europa.eu/en/medicines/human/orphan-designations/eu-3-18-2049